EMA orphan designation EU/3/12/981: Luxturna
Active substance: adenovirus-associated viral vector serotype 2 containing the human RPE65 gene (voretigene neparvovec)
Designated 2012-04-02. Orphan designation status: Positive. EMA product number: EMEA/H/C/004451.
First published: 2012-04-30. Last updated: 2019-03-04.

Intended use: Treatment of Leber's congenital amaurosis

Source: https://www.ema.europa.eu/en/medicines/human/orphan-designations/eu-3-12-981